EMA orphan designation EU/3/06/375
Active substance: nilotinib
Designated 2006-05-22. Orphan designation status: Expired.
First published: 2007-12-04. Last updated: 2020-01-20.

Intended use: Treatment of chronic myeloid leukaemia

Source: https://www.ema.europa.eu/en/medicines/human/orphan-designations/eu-3-06-375